EMA orphan designation EU/3/22/2745
Active substance: opelconazole
Designated 2023-01-13. Orphan designation status: Positive.
First published: 2023-03-23.

Intended use: Treatment of invasive aspergillosis

Source: https://www.ema.europa.eu/en/medicines/human/orphan-designations/eu-3-22-2745